EMA orphan designation EU/3/20/2384
Active substance: sulindac
Designated 2020-12-09. Orphan designation status: Positive.
First published: 2021-05-26. Last updated: 2021-10-12.

Intended use: Treatment of fragile X syndrome

Source: https://www.ema.europa.eu/en/medicines/human/orphan-designations/eu-3-20-2384